EMA orphan designation EU/3/08/549: Zafiride
Active substance: NGR-human tumour necrosis factor
Designated 2008-06-03. Orphan designation status: Positive. EMA product number: EMEA/H/C/004455.
First published: 2008-07-29. Last updated: 2008-07-29.

Intended use: Treatment of malignant mesothelioma

Source: https://www.ema.europa.eu/en/medicines/human/orphan-designations/eu-3-08-549